EMA orphan designation EU/3/02/104
Active substance: Miltefosine
Designated 2002-06-12. Orphan designation status: Positive.
First published: 2003-06-11. Last updated: 2003-06-11.

Intended use: Treatment of visceral leishmaniasis

Source: https://www.ema.europa.eu/en/medicines/human/orphan-designations/eu-3-02-104